EMA orphan designation EU/3/16/1619
Active substance: DNA plasmid encoding a recombinant fusion protein consisting of the extracellular domain of human TNFα p55 receptor linked to the human IgG1 Fc domain
Designated 2016-02-17. Orphan designation status: Positive.
First published: 2016-04-07. Last updated: 2020-09-29.

Intended use: Treatment of non-infectious uveitis

Source: https://www.ema.europa.eu/en/medicines/human/orphan-designations/eu-3-16-1619